EMA orphan designation EU/3/22/2583
Active substance: cannabidiol
Designated 2022-02-25. Orphan designation status: Positive.
First published: 2022-05-13. Last updated: 2023-03-07.

Intended use: Treatment of fragile X syndrome (FXS)

Source: https://www.ema.europa.eu/en/medicines/human/orphan-designations/eu-3-22-2583